EMA orphan designation EU/3/16/1689
Active substance: teriparatide
Designated 2016-06-27. Orphan designation status: Positive.
First published: 2016-07-26. Last updated: 2021-09-16.

Intended use: Treatment of hypoparathyroidism

Source: https://www.ema.europa.eu/en/medicines/human/orphan-designations/eu-3-16-1689